EMA orphan designation EU/3/22/2637
Active substance: human allogeneic keratinocytes
Designated 2022-06-21. Orphan designation status: Positive.
First published: 2022-09-22.

Intended use: Treatment of partial deep dermal and full thickness burns

Source: https://www.ema.europa.eu/en/medicines/human/orphan-designations/eu-3-22-2637